EMA orphan designation EU/3/14/1245
Active substance: pioglitazone
Designated 2014-02-19. Orphan designation status: Positive.
First published: 2014-04-02. Last updated: 2014-04-02.

Intended use: Treatment of adrenoleukodystrophy

Source: https://www.ema.europa.eu/en/medicines/human/orphan-designations/eu-3-14-1245